EMA orphan designation EU/3/06/355
Active substance: zosuquidar trihydrochloride
Designated 2006-02-17. Orphan designation status: Positive.
First published: 2009-04-24. Last updated: 2009-04-24.

Intended use: Treatment of acute myeloid leukaemia

Source: https://www.ema.europa.eu/en/medicines/human/orphan-designations/eu-3-06-355